EMA orphan designation EU/3/14/1354
Active substance: selinexor
Designated 2014-11-19. Orphan designation status: Positive.
First published: 2015-01-15. Last updated: 2026-05-11.

Intended use: Treatment of chronic lymphocytic leukaemia / small lymphocytic lymphoma

Source: https://www.ema.europa.eu/en/medicines/human/orphan-designations/eu-3-14-1354